EMA orphan designation EU/3/09/654
Active substance: Hypothiocyanite; lactoferrin
Designated 2009-07-24. Orphan designation status: Positive.
First published: 2009-09-11. Last updated: 2013-03-20.

Intended use: Treatment of cystic fibrosis

Source: https://www.ema.europa.eu/en/medicines/human/orphan-designations/eu-3-09-654